EMA orphan designation EU/3/03/137
Active substance: tositumomab
Designated 2003-02-14. Orphan designation status: Withdrawn.
First published: 2005-12-12. Last updated: 2015-05-09.

Intended use: Treatment of follicular lymphoma

Source: https://www.ema.europa.eu/en/medicines/human/orphan-designations/eu-3-03-137